EMA orphan designation EU/3/11/939
Active substance: Brentuximab vedotin
Designated 2012-01-11. Orphan designation status: Withdrawn.
First published: 2012-02-01. Last updated: 2024-06-26.

Intended use: Treatment of cutaneous T-cell lymphoma

Source: https://www.ema.europa.eu/en/medicines/human/orphan-designations/eu-3-11-939